EMA orphan designation EU/3/05/316
Active substance: 2-{4-[(5,6-Diphenylpyrazin-2-yl)(isopropyl)amino]butoxy}-N-(methylsulfonyl)acetamide
Designated 2005-08-26. Orphan designation status: Withdrawn.
First published: 2009-09-17. Last updated: 2018-02-12.

Intended use: Treatment of pulmonary arterial hypertension and chronic thromboembolic pulmonary hypertension

Source: https://www.ema.europa.eu/en/medicines/human/orphan-designations/eu-3-05-316